EMA orphan designation EU/3/13/1149
Active substance: human haemin
Designated 2013-07-17. Orphan designation status: Positive.
First published: 2013-08-22. Last updated: 2013-08-22.

Intended use: Prevention of ischaemia/reperfusion injury associated with solid organ transplantation

Source: https://www.ema.europa.eu/en/medicines/human/orphan-designations/eu-3-13-1149